EMA orphan designation EU/3/25/3122
Active substance: darovasertib
Designated 2025-08-22. Orphan designation status: Positive.
First published: 2026-04-10.

Intended use: Treatment of uveal melanoma

Source: https://www.ema.europa.eu/en/medicines/human/orphan-designations/eu-3-25-3122